EMA orphan designation EU/3/24/3005
Active substance: 4-(4-(2-(Diethylamino)ethoxy)phenyl)-1-(4-methoxybenzyl)-1H-1,2,3-triazol-5-amine
Designated 2024-12-13. Orphan designation status: Positive.
First published: 2025-01-15.

Intended use: Treatment of neurofibromatosis type 2

Source: https://www.ema.europa.eu/en/medicines/human/orphan-designations/eu-3-24-3005